EMA orphan designation EU/3/10/809
Active substance: nanoparticle albumin-bound paclitaxel
Designated 2010-11-26. Orphan designation status: Withdrawn.
First published: 2010-12-17. Last updated: 2013-03-11.

Intended use: Treatment of pancreatic cancer

Source: https://www.ema.europa.eu/en/medicines/human/orphan-designations/eu-3-10-809